EMA orphan designation EU/3/09/716
Active substance: Benzamide, 3-(2-imidazo[1,2-b]pyridazin-3-ylethynyl)-4-methyl-N-[4-[(4-methyl-1-piperazinyl)methyl]-3-(trifluoromethyl)phenyl] (ponatinib)
Designated 2010-02-02. Orphan designation status: Expired.
First published: 2010-03-04. Last updated: 2023-07-20.

Intended use: Treatment of chronic myeloid leukaemia

Source: https://www.ema.europa.eu/en/medicines/human/orphan-designations/eu-3-09-716